EMA orphan designation EU/3/09/720
Active substance: Lentiviral vector containing the human ABCA4 gene
Designated 2010-02-02. Orphan designation status: Positive.
First published: 2010-03-04. Last updated: 2023-03-13.

Intended use: Treatment of Stargardt's disease

Source: https://www.ema.europa.eu/en/medicines/human/orphan-designations/eu-3-09-720